EMA orphan designation EU/3/09/668
Active substance: human C1 inhibitor
Designated 2009-10-08. Orphan designation status: Withdrawn.
First published: 2009-10-20. Last updated: 2011-05-19.

Intended use: Treatment of angioedema caused by C1 inhibitor deficiency

Source: https://www.ema.europa.eu/en/medicines/human/orphan-designations/eu-3-09-668